EMA orphan designation EU/3/12/1075
Active substance: Cyclo(-gamma-aminobutyryl-L-phenylalanyl-L-tryptophanyl-D-tryptophanyl-L-lysyl-L-threonyl-L phenylalanyl-N-3-carboxypropyl)-glycine amide, acetate salt
Designated 2012-12-06. Orphan designation status: Positive.
First published: 2013-01-24. Last updated: 2024-10-11.

Intended use: Treatment of acromegaly

Source: https://www.ema.europa.eu/en/medicines/human/orphan-designations/eu-3-12-1075